EMA orphan designation EU/3/19/2138
Active substance: allogeneic anti-Epstein Barr virus cytotoxic T lymphocytes expanded ex vivo
Orphan designation status: Withdrawn.
First published: 2019-10-02. Last updated: 2021-07-21.

Intended use: Treatment of post-transplant lymphoproliferative disorder

Source: https://www.ema.europa.eu/en/medicines/human/orphan-designations/eu-3-19-2138